EMA orphan designation EU/3/22/2685
Active substance: allogeneic adult liver-derived stem cells
Designated 2022-08-10. Orphan designation status: Positive.
First published: 2022-12-15.

Intended use: Treatment of urea cycle disorders

Source: https://www.ema.europa.eu/en/medicines/human/orphan-designations/eu-3-22-2685